EMA orphan designation EU/3/21/2478
Active substance: adeno-associated virus vector serotype 1 containing the human GRN gene
Designated 2021-07-19. Orphan designation status: Positive.
First published: 2021-11-16.

Intended use: Treatment of frontotemporal dementia

Source: https://www.ema.europa.eu/en/medicines/human/orphan-designations/eu-3-21-2478